EMA orphan designation EU/3/05/275
Active substance: estradiol hemihydrate; progesterone
Designated 2005-04-11. Orphan designation status: Positive.
First published: 2006-01-04. Last updated: 2011-11-18.

Intended use: Bronchopulmonary dysplasia in premature neonates of less than 30 weeks of gestational age

Source: https://www.ema.europa.eu/en/medicines/human/orphan-designations/eu-3-05-275